EMA orphan designation EU/3/08/580
Active substance: filgrastim
Designated 2008-11-07. Orphan designation status: Positive.
First published: 2009-04-24. Last updated: 2015-03-26.

Intended use: Treatment of spinal cord injury

Source: https://www.ema.europa.eu/en/medicines/human/orphan-designations/eu-3-08-580